EMA orphan designation EU/3/06/349
Active substance: apomorphine hydrochloride
Designated 2006-02-16. Orphan designation status: Positive.
First published: 2006-08-24. Last updated: 2023-02-28.

Intended use: Treatment of off-periods in Parkinson's disease not responding to oral treatment

Source: https://www.ema.europa.eu/en/medicines/human/orphan-designations/eu-3-06-349